EMA orphan designation EU/3/15/1575
Active substance: humanised fusion protein consisting of extracellular domain of CD24 linked to IgG1 Fc domain
Designated 2015-11-11. Orphan designation status: Withdrawn.
First published: 2016-01-06. Last updated: 2026-05-26.

Intended use: Prevention of graft-versus-host disease

Source: https://www.ema.europa.eu/en/medicines/human/orphan-designations/eu-3-15-1575